EMA orphan designation EU/3/16/1620
Active substance: allogeneic fetal human retinal progenitor cells expanded ex vivo
Designated 2016-02-17. Orphan designation status: Positive.
First published: 2016-04-07. Last updated: 2021-10-13.

Intended use: Treatment of retinitis pigmentosa

Source: https://www.ema.europa.eu/en/medicines/human/orphan-designations/eu-3-16-1620